EMA orphan designation EU/3/16/1692
Active substance: 3-[4-(1H-imidazol-1-ylmethyl)phenyl]-5-(2-methylpropyl)thiophene-2-[(N-butyloxylcarbamate)-sulphonamide] sodium salt
Designated 2016-07-14. Orphan designation status: Positive.
First published: 2016-09-06. Last updated: 2022-12-08.

Intended use: Treatment of idiopathic pulmonary fibrosis

Source: https://www.ema.europa.eu/en/medicines/human/orphan-designations/eu-3-16-1692